EMA orphan designation EU/3/25/3071
Active substance: beta-lapachone
Designated 2025-06-20. Orphan designation status: Positive.
First published: 2025-07-25. Last updated: 2026-05-11.

Intended use: Treatment of primary sclerosing cholangitis

Source: https://www.ema.europa.eu/en/medicines/human/orphan-designations/eu-3-25-3071